EMA orphan designation EU/3/01/053
Active substance: beraprost sodium
Designated 2001-09-18. Orphan designation status: Withdrawn.
First published: 2006-01-04. Last updated: 2006-01-04.

Intended use: Treatment of pulmonary arterial hypertension and chronic thromboembolic pulmonary hypertension

Source: https://www.ema.europa.eu/en/medicines/human/orphan-designations/eu-3-01-053